EMA orphan designation EU/3/24/2924
Active substance: autologous induced pluripotent stem cells-derived thymic epithelial cells transduced with a lentiviral vector encoding forkhead box protein N1
Designated 2024-05-24. Orphan designation status: Positive.
First published: 2024-08-22.

Intended use: Treatment of DiGeorge syndrome

Source: https://www.ema.europa.eu/en/medicines/human/orphan-designations/eu-3-24-2924